EMA orphan designation EU/3/12/1065
Active substance: synthetic double-stranded siRNA oligonucleotide directed against claudin-5 complexed with polyethyleneimine
Designated 2012-11-08. Orphan designation status: Withdrawn.
First published: 2012-12-12. Last updated: 2017-05-17.

Intended use: Treatment of glioma

Source: https://www.ema.europa.eu/en/medicines/human/orphan-designations/eu-3-12-1065